EMA orphan designation EU/3/17/1889: Ayvakyt
Active substance: (S)-1-(4-fluorophenyl)-1-(2-(4-(6-(1-methyl-1H-pyrazol-4-yl)pyrrolo[2,1-f][1,2,4]triazin-4-yl)piperazin-yl)pyrimidin-5-yl)ethan-1-amine
Orphan designation status: Positive. EMA product number: EMEA/H/C/005208.
First published: 2017-07-14. Last updated: 2019-05-22.

Intended use: Treatment of gastrointestinal stromal tumours

Source: https://www.ema.europa.eu/en/medicines/human/orphan-designations/eu-3-17-1889